EMA orphan designation EU/3/12/1063
Active substance: panobinostat
Designated 2012-11-08. Orphan designation status: Expired.
First published: 2012-12-13. Last updated: 2025-09-16.

Intended use: Treatment of multiple myeloma

Source: https://www.ema.europa.eu/en/medicines/human/orphan-designations/eu-3-12-1063